EMA orphan designation EU/3/12/977
Active substance: Linsitinib
Designated 2012-04-02. Orphan designation status: Withdrawn.
First published: 2012-04-30. Last updated: 2013-03-11.

Intended use: Treatment of adrenal cortical carcinoma

Source: https://www.ema.europa.eu/en/medicines/human/orphan-designations/eu-3-12-977